EMA orphan designation EU/3/10/748
Active substance: pravastatin; zoledronic acid
Designated 2010-06-09. Orphan designation status: Positive.
First published: 2010-06-23. Last updated: 2010-06-23.

Intended use: Treatment of Hutchinson-Gilford progeria

Source: https://www.ema.europa.eu/en/medicines/human/orphan-designations/eu-3-10-748